EMA orphan designation EU/3/20/2298
Active substance: Hemopexin
Designated 2020-07-27. Orphan designation status: Positive.
First published: 2020-11-19.

Intended use: Treatment of sickle cell disease

Source: https://www.ema.europa.eu/en/medicines/human/orphan-designations/eu-3-20-2298